EMA orphan designation EU/3/16/1612
Active substance: Methyl 3-((2R)-2-hydroxy-4-(((((S)-1-methoxy-1-oxopropan-2-yl) amino)(phenoxy)phosphoryl)oxy)-3,3-dimethylbutanamido)propanoate
Designated 2016-02-17. Orphan designation status: Withdrawn.
First published: 2016-04-06. Last updated: 2020-08-13.

Intended use: Treatment of pantothenate-kinase-associated neurodegeneration

Source: https://www.ema.europa.eu/en/medicines/human/orphan-designations/eu-3-16-1612